EMA orphan designation EU/3/26/3206
Active substance: rinatabart sesutecan
Orphan designation status: Positive.
First published: 2026-04-14.

Intended use: Treatment of ovarian cancer

Source: https://www.ema.europa.eu/en/medicines/human/orphan-designations/eu-3-26-3206